EMA orphan designation EU/3/19/2144
Active substance: Poly(N-acetyl, N-arginyl)glucosamine
Designated 2019-02-26. Orphan designation status: Positive.
First published: 2019-04-09.

Intended use: Treatment of cystic fibrosis

Source: https://www.ema.europa.eu/en/medicines/human/orphan-designations/eu-3-19-2144